EMA orphan designation EU/3/12/1035
Active substance: recombinant human monoclonal antibody against activin receptor type IIB
Designated 2012-08-09. Orphan designation status: Withdrawn.
First published: 2012-09-27. Last updated: 2018-10-24.

Intended use: Treatment of inclusion body myositis

Source: https://www.ema.europa.eu/en/medicines/human/orphan-designations/eu-3-12-1035